EMA orphan designation EU/3/14/1364
Active substance: olaptesed pegol
Designated 2014-11-19. Orphan designation status: Positive.
First published: 2015-01-15. Last updated: 2015-01-15.

Intended use: Treatment of glioma

Source: https://www.ema.europa.eu/en/medicines/human/orphan-designations/eu-3-14-1364